EMA orphan designation EU/3/20/2329: Febseltiq
Active substance: Infigratinib
Designated 2020-08-21. Orphan designation status: Withdrawn. EMA product number: EMEA/H/C/005361.
First published: 2020-11-25. Last updated: 2022-11-08.

Intended use: Treatment of cholangiocarcinoma

Source: https://www.ema.europa.eu/en/medicines/human/orphan-designations/eu-3-20-2329